EMA orphan designation EU/3/07/455
Active substance: ciclosporin
Designated 2007-10-22. Orphan designation status: Withdrawn.
First published: 2008-07-02. Last updated: 2015-05-13.

Intended use: Prevention of corneal graft rejection

Source: https://www.ema.europa.eu/en/medicines/human/orphan-designations/eu-3-07-455